EMA orphan designation EU/3/07/462
Active substance: recombinant human soluble Fc-gamma receptor II b
Designated 2007-08-02. Orphan designation status: Withdrawn.
First published: 2008-07-29. Last updated: 2019-10-16.

Intended use: Treatment of idiopathic thrombocytopenic purpura

Source: https://www.ema.europa.eu/en/medicines/human/orphan-designations/eu-3-07-462